EMA orphan designation EU/3/18/2095
Active substance: Ile-Ser-Ile-Thr-Glu-Ile-Lys-Gly-Val-Ile-Val-His-Arg-Ile-Glu-Thr-Ile-Leu-Phe-Lys-Lys-Lys-Lys-Glu-Met-Pro-Ser-Glu-Glu-Gly-Tyr-Gln-Asp
Designated 2018-11-19. Orphan designation status: Positive.
First published: 2019-02-25. Last updated: 2023-04-28.

Intended use: Treatment of multiple system atrophy

Source: https://www.ema.europa.eu/en/medicines/human/orphan-designations/eu-3-18-2095